EMA orphan designation EU/3/04/236
Active substance: recombinant human insulin-like growth factor-I; recombinant human insulin-like growth factor binding protein-3
Designated 2004-10-21. Orphan designation status: Withdrawn.
First published: 2011-06-21. Last updated: 2011-06-21.

Intended use: Treatment of type A extreme insulin resistance syndrome

Source: https://www.ema.europa.eu/en/medicines/human/orphan-designations/eu-3-04-236